EMA orphan designation EU/3/19/2139
Active substance: autologous adult live cultured osteoblasts
Designated 2019-02-26. Orphan designation status: Positive.
First published: 2019-04-09. Last updated: 2020-08-27.

Intended use: Treatment of non-traumatic osteonecrosis

Source: https://www.ema.europa.eu/en/medicines/human/orphan-designations/eu-3-19-2139